EMA orphan designation EU/3/16/1808
Active substance: (6aR, 10aR)-3-(1',1'-dimethylheptyl)-delta-8-tetrahydro-cannabinol-9-carboxylic acid
Designated 2017-01-12. Orphan designation status: Withdrawn.
First published: 2017-07-14. Last updated: 2024-04-09.

Intended use: Treatment of systemic sclerosis

Source: https://www.ema.europa.eu/en/medicines/human/orphan-designations/eu-3-16-1808